EMA orphan designation EU/3/10/776
Active substance: autologous dendritic cells pulsed with recombinant human-fusion protein (mucin 1 - glutathione S transferase) coupled to oxidised polymannose
Designated 2010-09-20. Orphan designation status: Positive.
First published: 2010-10-18. Last updated: 2013-10-17.

Intended use: Treatment of ovarian cancer

Source: https://www.ema.europa.eu/en/medicines/human/orphan-designations/eu-3-10-776